EMA orphan designation EU/3/20/2256
Active substance: reldesemtiv
Designated 2020-02-28. Orphan designation status: Positive.
First published: 2020-05-06. Last updated: 2024-10-11.

Intended use: Treatment of amyotrophic lateral sclerosis

Source: https://www.ema.europa.eu/en/medicines/human/orphan-designations/eu-3-20-2256